EMA orphan designation EU/3/19/2184: Ezmekly
Active substance: N-((R)-2,3-dihydroxypropoxyl)-3,4-difluro-2-(2-fluoro-4-iodo-phenylamino)-benzamide
Designated 2019-07-25. Orphan designation status: Positive. EMA product number: EMEA/H/C/006460.
First published: 2019-09-24. Last updated: 2024-04-11.

Intended use: Treatment of neurofibromatosis type 1

Source: https://www.ema.europa.eu/en/medicines/human/orphan-designations/eu-3-19-2184